EMA orphan designation EU/3/17/1911
Active substance: sodium 2-hydroxylinoleate
Designated 2017-08-23. Orphan designation status: Positive.
First published: 2017-10-17. Last updated: 2017-10-17.

Intended use: Treatment of pancreatic cancer

Source: https://www.ema.europa.eu/en/medicines/human/orphan-designations/eu-3-17-1911